EMA orphan designation EU/3/25/3046
Active substance: vamikibart
Designated 2025-04-16. Orphan designation status: Positive.
First published: 2025-06-05.

Intended use: Treatment of non-infectious uveitis

Source: https://www.ema.europa.eu/en/medicines/human/orphan-designations/eu-3-25-3046